EMA orphan designation EU/3/14/1237
Active substance: Gallium [Ga-68]-N-[(4,7,10-Tricarboxymethyl-1,4,7,10-tetraazacyclododec-1-yl)acetyl]-D-phenylalanyl-L-cysteinyl-L-tyrosyl-D-tryptophanyl-L-lysyl-L-threoninyl-Lcysteinyl-L-threonine-cyclic(2-7)disulfide
Designated 2014-02-19. Orphan designation status: Positive.
First published: 2014-04-01. Last updated: 2023-05-03.

Intended use: Diagnosis of gastro-entero-pancreatic neuroendocrine tumours

Source: https://www.ema.europa.eu/en/medicines/human/orphan-designations/eu-3-14-1237